EMA orphan designation EU/3/18/2066: Pemazyre
Active substance: pemigatinib
Designated 2018-08-24. Orphan designation status: Positive. EMA product number: EMEA/H/C/005266.
First published: 2018-12-19. Last updated: 2021-05-04.

Intended use: Treatment of biliary tract cancer

Source: https://www.ema.europa.eu/en/medicines/human/orphan-designations/eu-3-18-2066